EMA orphan designation EU/3/16/1662
Active substance: H-Phe-Ser-Arg-Tyr-Ala-Arg-OH acetate (Alirinetide)
Designated 2016-05-30. Orphan designation status: Positive.
First published: 2016-06-22. Last updated: 2018-12-18.

Intended use: Treatment of amyotrophic lateral sclerosis

Source: https://www.ema.europa.eu/en/medicines/human/orphan-designations/eu-3-16-1662